EMA orphan designation EU/3/15/1548
Active substance: ovine specific immunoglobulin (Fab) fragments raised against Vipera berus veno
Designated 2015-10-09. Orphan designation status: Positive.
First published: 2015-11-16. Last updated: 2022-10-21.

Intended use: Treatment of snakebite envenomation

Source: https://www.ema.europa.eu/en/medicines/human/orphan-designations/eu-3-15-1548